EMA orphan designation EU/3/22/2617
Active substance: autologous peripheral blood-derived CD4 T-cells CRISPR-edited at the CD40LG locus
Designated 2022-05-16. Orphan designation status: Positive.
First published: 2022-12-14.

Intended use: Treatment of hyper IgM syndromes

Source: https://www.ema.europa.eu/en/medicines/human/orphan-designations/eu-3-22-2617